EMA orphan designation EU/3/06/369
Active substance: bilayer engineered skin composed of keratinocytes from the patient (autologous) and fibroblasts from a donor (allogeneic) embedded in a plasma matrix
Designated 2006-05-22. Orphan designation status: Positive.
First published: 2009-05-15. Last updated: 2015-03-11.

Intended use: Treatment of epidermolysis bullosa

Source: https://www.ema.europa.eu/en/medicines/human/orphan-designations/eu-3-06-369